EMA orphan designation EU/3/24/3017
Active substance: volixibat potassium
Designated 2025-01-16. Orphan designation status: Positive.
First published: 2025-02-20.

Intended use: Treatment of primary biliary cholangitis

Source: https://www.ema.europa.eu/en/medicines/human/orphan-designations/eu-3-24-3017